EMA orphan designation EU/3/26/3219
Active substance: dusquetide
Designated 2026-03-25. Orphan designation status: Positive.
First published: 2026-04-20.

Intended use: Treatment of Behçet's Disease

Source: https://www.ema.europa.eu/en/medicines/human/orphan-designations/eu-3-26-3219